EMA orphan designation EU/3/21/2465
Active substance: Eftansomatropin alfa
Designated 2021-07-19. Orphan designation status: Positive.
First published: 2021-11-12.

Intended use: Treatment of growth hormone deficiency

Source: https://www.ema.europa.eu/en/medicines/human/orphan-designations/eu-3-21-2465